EMA orphan designation EU/3/16/1789
Active substance: synthetic human hepcidin
Designated 2016-11-18. Orphan designation status: Withdrawn.
First published: 2016-12-13. Last updated: 2022-05-18.

Intended use: Treatment of sickle cell disease

Source: https://www.ema.europa.eu/en/medicines/human/orphan-designations/eu-3-16-1789